EMA orphan designation EU/3/07/497
Active substance: 4-[3,5-Bis(trimethylsilyl)benzamido] benzoic acid
Designated 2007-10-22. Orphan designation status: Withdrawn.
First published: 2008-07-29. Last updated: 2011-05-18.

Intended use: Treatment of hepatocellular carcinoma

Source: https://www.ema.europa.eu/en/medicines/human/orphan-designations/eu-3-07-497